EMA orphan designation EU/3/17/1912
Active substance: tacrolimus
Designated 2017-08-23. Orphan designation status: Positive.
First published: 2017-10-17. Last updated: 2017-10-17.

Intended use: Treatment of pulmonary arterial hypertension

Source: https://www.ema.europa.eu/en/medicines/human/orphan-designations/eu-3-17-1912